EMA orphan designation EU/3/21/2500
Active substance: Adeno-associated virus of serotype rh10 encoding Human MLC1 under the control of GFAP promoter
Designated 2021-10-15. Orphan designation status: Positive.
First published: 2022-01-20. Last updated: 2022-11-11.

Intended use: Treatment of megalencephalic leukoencephalopathy with subcortical cysts

Source: https://www.ema.europa.eu/en/medicines/human/orphan-designations/eu-3-21-2500